EMA orphan designation EU/3/07/439
Active substance: recombinant human monoclonal antibody to human IL-1beta of the IgG1/K class
Designated 2007-03-20. Orphan designation status: Withdrawn.
First published: 2009-04-08. Last updated: 2015-02-12.

Intended use: Treatment of cryopirin-associated periodic syndromes (FCUS, MWS, and NOMID)

Source: https://www.ema.europa.eu/en/medicines/human/orphan-designations/eu-3-07-439